EMA orphan designation EU/3/13/1161
Active substance: heterologous human adult liver-derived progenitor cells
Designated 2013-07-17. Orphan designation status: Positive.
First published: 2013-08-21. Last updated: 2024-10-15.

Intended use: Treatment of carbamoyl-phosphate synthase-1 deficiency

Source: https://www.ema.europa.eu/en/medicines/human/orphan-designations/eu-3-13-1161